EMA orphan designation EU/3/17/1928
Active substance: recombinant adeno-associated viral vector serotype 5 encoding Staphylococcus aureus Cas9 endonuclease and two guide RNAs complementary to two regions of intron 26 of the CEP290 gene
Designated 2017-10-16. Orphan designation status: Withdrawn.
First published: 2018-01-08. Last updated: 2025-06-05.

Intended use: Treatment of Leber's congenital amaurosis

Source: https://www.ema.europa.eu/en/medicines/human/orphan-designations/eu-3-17-1928